EMA orphan designation EU/3/24/2997
Active substance: Colistimethate sodium
Designated 2024-12-13. Orphan designation status: Positive.
First published: 2025-01-15.

Intended use: Treatment of cystic fibrosis

Source: https://www.ema.europa.eu/en/medicines/human/orphan-designations/eu-3-24-2997